EMA orphan designation EU/3/09/711
Active substance: recombinant human vascular endothelial growth factor
Designated 2010-01-29. Orphan designation status: Positive.
First published: 2010-02-25. Last updated: 2014-05-12.

Intended use: Treatment of amyotrophic lateral sclerosis

Source: https://www.ema.europa.eu/en/medicines/human/orphan-designations/eu-3-09-711